EMA orphan designation EU/3/01/082
Active substance: 2-Chloro-9-[2-deoxy-2-fluoro-ß-D-arabinofuranosyl]adenine
Designated 2002-02-05. Orphan designation status: Expired.
First published: 2008-08-18. Last updated: 2016-08-10.

Intended use: Treatment of acute lymphoblastic leukaemia

Source: https://www.ema.europa.eu/en/medicines/human/orphan-designations/eu-3-01-082